EMA orphan designation EU/3/25/3058
Active substance: tranilast
Designated 2025-05-22. Orphan designation status: Positive.
First published: 2025-06-25.

Intended use: Treatment of idiopathic pulmonary fibrosis

Source: https://www.ema.europa.eu/en/medicines/human/orphan-designations/eu-3-25-3058